EMA orphan designation EU/3/22/2647
Active substance: autologous naive regulatory T cells transduced with a lentiviral vector encoding for a chimeric antigen receptor to recognise the HLA-A*02 antigen
Designated 2022-07-18. Orphan designation status: Positive.
First published: 2023-01-25.

Intended use: Treatment in solid organ transplantation

Source: https://www.ema.europa.eu/en/medicines/human/orphan-designations/eu-3-22-2647